EMA orphan designation EU/3/15/1586: Oxervate
Active substance: recombinant human nerve growth factor (cenegermin)
Designated 2015-12-14. Orphan designation status: Positive. EMA product number: EMEA/H/C/004209.
First published: 2016-02-02. Last updated: 2016-02-02.

Intended use: Treatment of neurotrophic keratitis

Source: https://www.ema.europa.eu/en/medicines/human/orphan-designations/eu-3-15-1586